EMA orphan designation EU/3/00/017
Active substance: arsenic trioxide
Designated 2001-01-17. Orphan designation status: Withdrawn.
First published: 2009-05-15. Last updated: 2009-05-15.

Intended use: Treatment of acute promyelocytic leukaemia

Source: https://www.ema.europa.eu/en/medicines/human/orphan-designations/eu-3-00-017